EMA orphan designation EU/3/02/098
Active substance: Epothilone B
Designated 2002-03-22. Orphan designation status: Withdrawn.
First published: 2003-06-11. Last updated: 2015-02-09.

Intended use: Treatment of ovarian cancer

Source: https://www.ema.europa.eu/en/medicines/human/orphan-designations/eu-3-02-098